EMA orphan designation EU/3/25/3040
Active substance: deucrictibant monohydrate
Designated 2025-03-25. Orphan designation status: Positive.
First published: 2025-04-04.

Intended use: Treatment of bradykinin-mediated angioedema

Source: https://www.ema.europa.eu/en/medicines/human/orphan-designations/eu-3-25-3040